EMA orphan designation EU/3/08/595
Active substance: monoclonal antibody against human CD30 covalently linked to the cytotoxin monomethylauristatin E
Orphan designation status: Withdrawn.
First published: 2009-05-15. Last updated: 2024-06-26.

Intended use: Treatment of peripheral T-cell lymphoma

Source: https://www.ema.europa.eu/en/medicines/human/orphan-designations/eu-3-08-595